EMA orphan designation EU/3/15/1544
Active substance: 2-(2-phenylvinyl)-4-[4- methylpiperazin-1-yl)]-6-(5-methyl-2H-pyrazol-3-yl-amino)-pyrimidine L(+) tartrate salt
Designated 2015-08-10. Orphan designation status: Positive.
First published: 2015-10-02. Last updated: 2022-03-18.

Intended use: Treatment of hepatocellular carcinoma

Source: https://www.ema.europa.eu/en/medicines/human/orphan-designations/eu-3-15-1544